EMA orphan designation EU/3/23/2753
Active substance: patidistrogene bexoparvovec
Designated 2023-02-15. Orphan designation status: Positive.
First published: 2024-01-25.

Intended use: Treatment of limb girdle muscular dystrophy

Source: https://www.ema.europa.eu/en/medicines/human/orphan-designations/eu-3-23-2753